EMA orphan designation EU/3/25/3041
Active substance: allopurinol
Designated 2025-03-25. Orphan designation status: Positive.
First published: 2025-04-07.

Intended use: Treatment of Marfan syndrome

Source: https://www.ema.europa.eu/en/medicines/human/orphan-designations/eu-3-25-3041